EMA orphan designation EU/3/05/269
Active substance: tipifarnib
Designated 2005-03-10. Orphan designation status: Withdrawn.
First published: 2005-06-01. Last updated: 2026-05-18.

Intended use: Treatment of acute myeloid leukaemia

Source: https://www.ema.europa.eu/en/medicines/human/orphan-designations/eu-3-05-269